EMA orphan designation EU/3/10/820: Heparesc
Active substance: human heterologous liver cells
Designated 2010-12-17. Orphan designation status: Positive. EMA product number: EMEA/H/C/003750.
First published: 2011-01-20. Last updated: 2016-08-16.

Intended use: Treatment of argininosuccinic aciduria

Source: https://www.ema.europa.eu/en/medicines/human/orphan-designations/eu-3-10-820